EMA orphan designation EU/3/14/1340
Active substance: cultured allogeneic corneal limbal stem cells
Designated 2014-10-15. Orphan designation status: Positive.
First published: 2014-11-12. Last updated: 2014-11-12.

Intended use: Treatment of limbal stem cell deficiency

Source: https://www.ema.europa.eu/en/medicines/human/orphan-designations/eu-3-14-1340